EMA orphan designation EU/3/24/2954
Active substance: sodium phenylacetate; ursodoxicoltaurine
Designated 2024-07-25. Orphan designation status: Positive.
First published: 2024-09-13.

Intended use: Treatment of Wolfram syndrome

Source: https://www.ema.europa.eu/en/medicines/human/orphan-designations/eu-3-24-2954